EMA orphan designation EU/3/22/2624
Active substance: Doxorubicin
Designated 2022-06-21. Orphan designation status: Positive.
First published: 2022-09-15.

Intended use: Treatment of soft tissue sarcoma

Source: https://www.ema.europa.eu/en/medicines/human/orphan-designations/eu-3-22-2624